EMA orphan designation EU/3/15/1472
Active substance: fluciclovine (18F)
Designated 2015-04-24. Orphan designation status: Positive.
First published: 2015-05-21. Last updated: 2015-05-21.

Intended use: Diagnosis of glioma

Source: https://www.ema.europa.eu/en/medicines/human/orphan-designations/eu-3-15-1472